EMA orphan designation EU/3/20/2350: Yorvipath
Active substance: Poly(oxy-1,2-ethanediyl), alpha-hydro-omega-methoxy, ether with N-[[[2-[[6-[[1-[3-[[3-(2,3-dihydroxypropoxy)propyl]amino]-3-oxopropyl]-2,5-dioxo-3-pyrrolidinyl]thio]hexyl]amino]ethyl]amino]carbonyl]-2-methylalanyl-teriparatide (2:1)
Designated 2020-10-19. Orphan designation status: Positive. EMA product number: EMEA/H/C/005934.
First published: 2021-02-18. Last updated: 2023-09-15.

Intended use: Treatment of hypoparathyroidism

Source: https://www.ema.europa.eu/en/medicines/human/orphan-designations/eu-3-20-2350